EMA orphan designation EU/3/14/1322
Active substance: Humanised IgG1 monoclonal antibody against human KIR3DL2
Designated 2014-08-22. Orphan designation status: Positive.
First published: 2014-10-01. Last updated: 2014-10-01.

Intended use: Treatment of cutaneous T-cell lymphoma

Source: https://www.ema.europa.eu/en/medicines/human/orphan-designations/eu-3-14-1322